EMA orphan designation EU/3/24/2996
Active substance: divesiran
Designated 2024-12-13. Orphan designation status: Positive.
First published: 2025-01-15.

Intended use: Treatment of polycythaemia vera

Source: https://www.ema.europa.eu/en/medicines/human/orphan-designations/eu-3-24-2996